EMA orphan designation EU/3/21/2555
Active substance: efgartigimod alfa
Designated 2022-01-14. Orphan designation status: Positive.
First published: 2022-06-09.

Intended use: Treatment of chronic inflammatory demyelinating polyneuropathy

Source: https://www.ema.europa.eu/en/medicines/human/orphan-designations/eu-3-21-2555